EMA orphan designation EU/3/08/604
Active substance: recombinant human minibody against complement component C5 fused with RGD-motif
Designated 2009-01-20. Orphan designation status: Withdrawn.
First published: 2009-06-29. Last updated: 2019-04-12.

Intended use: Prevention of ischaemia/reperfusion injury associated with solid organ transplantation

Source: https://www.ema.europa.eu/en/medicines/human/orphan-designations/eu-3-08-604